EMA orphan designation EU/3/05/296
Active substance: hydrocortisone
Designated 2005-07-27. Orphan designation status: Positive.
First published: 2009-09-21. Last updated: 2021-06-14.

Intended use: Treatment of congenital adrenal hyperplasia

Source: https://www.ema.europa.eu/en/medicines/human/orphan-designations/eu-3-05-296